EMA orphan designation EU/3/08/529
Active substance: tretazicar
Designated 2008-02-04. Orphan designation status: Positive.
First published: 2008-07-10. Last updated: 2013-07-08.

Intended use: Treatment of visceral leishmaniasis

Source: https://www.ema.europa.eu/en/medicines/human/orphan-designations/eu-3-08-529